EMA orphan designation EU/3/18/2077
Active substance: melatonin
Designated 2018-10-26. Orphan designation status: Positive.
First published: 2018-12-19.

Intended use: Treatment of acute radiation syndrome

Source: https://www.ema.europa.eu/en/medicines/human/orphan-designations/eu-3-18-2077